EMA orphan designation EU/3/18/2118: Zokinvy
Active substance: Lonafarnib
Designated 2018-12-14. Orphan designation status: Positive. EMA product number: EMEA/H/C/005271.
First published: 2019-03-11. Last updated: 2019-08-01.

Intended use: Treatment of Hutchinson-Gilford progeria

Source: https://www.ema.europa.eu/en/medicines/human/orphan-designations/eu-3-18-2118